EMA orphan designation EU/3/19/2232
Active substance: Lactobacillus plantarum
Designated 2019-12-16. Orphan designation status: Withdrawn.
First published: 2020-02-25. Last updated: 2025-04-09.

Intended use: Treatment of amyotrophic lateral sclerosis

Source: https://www.ema.europa.eu/en/medicines/human/orphan-designations/eu-3-19-2232